EMA orphan designation EU/3/12/986
Active substance: Pomalidomide
Designated 2012-04-26. Orphan designation status: Withdrawn.
First published: 2012-06-18. Last updated: 2015-06-08.

Intended use: Treatment of systemic sclerosis

Source: https://www.ema.europa.eu/en/medicines/human/orphan-designations/eu-3-12-986